EMA orphan designation EU/3/12/961
Active substance: Doxycycline hyclate
Designated 2012-03-05. Orphan designation status: Positive.
First published: 2012-04-19. Last updated: 2012-04-19.

Intended use: Treatment of systemic amyloidosis caused by beta-2 microglobulin

Source: https://www.ema.europa.eu/en/medicines/human/orphan-designations/eu-3-12-961